EMA orphan designation EU/3/12/968
Active substance: human monoclonal antibody targeting Staphylococcus aureus alpha-toxin (tosatoxumab)
Designated 2012-03-05. Orphan designation status: Positive.
First published: 2012-07-06. Last updated: 2021-07-13.

Intended use: Treatment of pneumonia caused by Staphylococcus aureus

Source: https://www.ema.europa.eu/en/medicines/human/orphan-designations/eu-3-12-968